EMA orphan designation EU/3/04/254
Active substance: Val-Leu-Gln-Glu-Leu-Asn-Val-Thr-Val (Pr1 nanopeptide, sequence 169-177, of proteinase 3)
Designated 2004-12-21. Orphan designation status: Withdrawn.
First published: 2006-01-06. Last updated: 2006-01-06.

Intended use: Treatment of myelodysplastic syndromes

Source: https://www.ema.europa.eu/en/medicines/human/orphan-designations/eu-3-04-254